EMA orphan designation EU/3/21/2548
Active substance: cedazuridine; decitabine
Designated 2021-12-10. Orphan designation status: Withdrawn.
First published: 2022-04-28. Last updated: 2023-07-06.

Intended use: Treatment of acute myeloid leukaemia

Source: https://www.ema.europa.eu/en/medicines/human/orphan-designations/eu-3-21-2548